EMA orphan designation EU/3/24/3004
Active substance: monepantel
Designated 2024-12-13. Orphan designation status: Positive.
First published: 2025-01-15.

Intended use: Treatment of amyotrophic lateral sclerosis

Source: https://www.ema.europa.eu/en/medicines/human/orphan-designations/eu-3-24-3004